EMA orphan designation EU/3/25/3050
Active substance: small-interfering RNA against soluble fms-like tyrosine kinase-1 e15a mRNA, small-interfering RNA against soluble fms-like tyrosine kinase-1 i13 mRNA
Designated 2025-04-16. Orphan designation status: Positive.
First published: 2025-06-05.

Intended use: Treatment of pre-eclampsia

Source: https://www.ema.europa.eu/en/medicines/human/orphan-designations/eu-3-25-3050